EMA orphan designation EU/3/24/2963
Active substance: Polihexanide
Designated 2024-08-21. Orphan designation status: Positive.
First published: 2024-09-18.

Intended use: Treatment of fungal keratitis

Source: https://www.ema.europa.eu/en/medicines/human/orphan-designations/eu-3-24-2963